EMA orphan designation EU/3/17/1874
Active substance: recombinant adeno-associated viral vector serotype 6 encoding the B-domain-deleted human factor VIII
Designated 2017-05-22. Orphan designation status: Positive.
First published: 2017-06-29. Last updated: 2019-07-30.

Intended use: Treatment of haemophilia A

Source: https://www.ema.europa.eu/en/medicines/human/orphan-designations/eu-3-17-1874